EMA orphan designation EU/3/04/248
Active substance: recombinant histidine-tagged idiotype immunoglobulin Fab fragment of clonal B-cell receptors
Designated 2004-12-21. Orphan designation status: Positive.
First published: 2006-01-06. Last updated: 2011-06-01.

Intended use: Treatment of multiple myeloma

Source: https://www.ema.europa.eu/en/medicines/human/orphan-designations/eu-3-04-248